EMA orphan designation EU/3/15/1465
Active substance: ex-vivo-expanded autologous human keratinocytes containing epidermal stem cells transduced with a LAMB3-encoding retroviral vector
Designated 2015-03-19. Orphan designation status: Positive.
First published: 2015-05-11. Last updated: 2015-05-11.

Intended use: Treatment of epidermolysis bullosa

Source: https://www.ema.europa.eu/en/medicines/human/orphan-designations/eu-3-15-1465